EMA orphan designation EU/3/12/1085
Active substance: voclosporin
Designated 2012-12-06. Orphan designation status: Positive.
First published: 2013-01-25. Last updated: 2013-01-25.

Intended use: Treatment of non-infectious uveitis

Source: https://www.ema.europa.eu/en/medicines/human/orphan-designations/eu-3-12-1085